EMA orphan designation EU/3/07/517
Active substance: N-[4-(3-amino-1H-indazol-4 yl)phenyl]-N'-(2-fluoro-5-methylphenyl) urea
Designated 2007-12-20. Orphan designation status: Withdrawn.
First published: 2008-07-02. Last updated: 2016-08-19.

Intended use: Treatment of hepatocellular carcinoma

Source: https://www.ema.europa.eu/en/medicines/human/orphan-designations/eu-3-07-517